EMA orphan designation EU/3/13/1197
Active substance: autologous ex-vivo-expanded leucocytes treated with 5-aza-2'-deoxycytidine
Designated 2013-11-13. Orphan designation status: Positive.
First published: 2013-11-28. Last updated: 2013-11-28.

Intended use: Treatment of glioma

Source: https://www.ema.europa.eu/en/medicines/human/orphan-designations/eu-3-13-1197